EMA orphan designation EU/3/24/2938
Active substance: uridine triacetate
Designated 2024-06-28. Orphan designation status: Positive.
First published: 2024-08-22. Last updated: 2024-08-23.

Intended use: Treatment of hereditary orotic aciduria

Source: https://www.ema.europa.eu/en/medicines/human/orphan-designations/eu-3-24-2938